EMA orphan designation EU/3/08/533
Active substance: recombinant human monoclonal antibody against transforming growth factor beta-1, 2 and 3
Designated 2008-04-01. Orphan designation status: Withdrawn.
First published: 2008-07-10. Last updated: 2016-01-19.

Intended use: Treatment of idiopathic pulmonary fibrosis

Source: https://www.ema.europa.eu/en/medicines/human/orphan-designations/eu-3-08-533